EMA orphan designation EU/3/18/2016
Active substance: adeno-associated viral vector serotype 9 containing the human CLN1 gene
Designated 2018-05-25. Orphan designation status: Positive.
First published: 2018-07-23. Last updated: 2018-07-23.

Intended use: Treatment of neuronal ceroid lipofuscinosis

Source: https://www.ema.europa.eu/en/medicines/human/orphan-designations/eu-3-18-2016